EMA orphan designation EU/3/20/2363
Active substance: 3,5-diamino-6-chloro-N-(N-(4-(4-(2-(hexyl((2S,3R,4R,5R)-2,3,4,5,6-pentahydroxyhexyl)amino)ethoxy)phenyl)butyl)-carbamimidoyl)pyrazine-2-carboxamide, Sodium chloride solution 4.2% (w/v)
Designated 2020-11-16. Orphan designation status: Positive.
First published: 2021-02-15. Last updated: 2023-03-07.

Intended use: Treatment of primary ciliary dyskinesia

Source: https://www.ema.europa.eu/en/medicines/human/orphan-designations/eu-3-20-2363